EMA orphan designation EU/3/06/397
Active substance: cholest-4-en-3-one, oxime
Designated 2006-08-28. Orphan designation status: Withdrawn.
First published: 2009-04-24. Last updated: 2013-10-17.

Intended use: Treatment of amyotrophic lateral sclerosis

Source: https://www.ema.europa.eu/en/medicines/human/orphan-designations/eu-3-06-397